EMA orphan designation EU/3/21/2482
Active substance: adeno-associated viral vector serotype 9 containing the human HEXA and HEXB genes
Designated 2021-08-20. Orphan designation status: Positive.
First published: 2022-02-25.

Intended use: Treatment of GM2 gangliosidosis

Source: https://www.ema.europa.eu/en/medicines/human/orphan-designations/eu-3-21-2482